EMA orphan designation EU/3/08/611
Active substance: recombinant human hepatocarcinoma-intestine-pancreas; pancreatic associated protein
Designated 2009-02-11. Orphan designation status: Positive.
First published: 2009-06-29. Last updated: 2021-10-13.

Intended use: Treatment of acute liver failure

Source: https://www.ema.europa.eu/en/medicines/human/orphan-designations/eu-3-08-611